EMA orphan designation EU/3/24/2958
Active substance: N,N'-([Cyclohexylmethylene]di-4,1-phenylene)bis(2-[1-pyrrolidinyl]acetamide)
Designated 2024-07-25. Orphan designation status: Positive.
First published: 2024-09-13.

Intended use: Treatment of Creutzfeldt-Jakob disease

Source: https://www.ema.europa.eu/en/medicines/human/orphan-designations/eu-3-24-2958